EMA orphan designation EU/3/21/2413
Active substance: adeno-associated viral vector serotype 9 expressing codon-optimized human GBA gene
Designated 2021-02-19. Orphan designation status: Positive.
First published: 2022-03-02.

Intended use: Treatment of Gaucher disease

Source: https://www.ema.europa.eu/en/medicines/human/orphan-designations/eu-3-21-2413